EMA orphan designation EU/3/08/537
Active substance: autologous urothelial and smooth muscle cells
Designated 2008-03-18. Orphan designation status: Withdrawn.
First published: 2008-07-10. Last updated: 2012-05-24.

Intended use: Treatment of spina bifida

Source: https://www.ema.europa.eu/en/medicines/human/orphan-designations/eu-3-08-537